EMA orphan designation EU/3/14/1235
Active substance: mixture of recombinant human IgG1 monoclonal antibodies against human cytomegalovirus envelope glycoproteins
Designated 2014-02-20. Orphan designation status: Withdrawn.
First published: 2014-04-01. Last updated: 2015-05-08.

Intended use: Prevention of congenital cytomegalovirus infection following primary cytomegalovirus infection

Source: https://www.ema.europa.eu/en/medicines/human/orphan-designations/eu-3-14-1235